EMA orphan designation EU/3/14/1351: Crysvita
Active substance: recombinant human monoclonal IgG1 antibody for fibroblast growth factor 23 (burosumab)
Designated 2014-10-15. Orphan designation status: Positive. EMA product number: EMEA/H/C/004275.
First published: 2014-11-12. Last updated: 2018-10-22.

Intended use: Treatment of X-linked hypophosphataemia

Source: https://www.ema.europa.eu/en/medicines/human/orphan-designations/eu-3-14-1351